EMA orphan designation EU/3/14/1370
Active substance: siponimod
Designated 2014-11-19. Orphan designation status: Withdrawn.
First published: 2015-01-16. Last updated: 2018-03-12.

Intended use: Treatment of polymyositis

Source: https://www.ema.europa.eu/en/medicines/human/orphan-designations/eu-3-14-1370